EMA orphan designation EU/3/21/2523
Active substance: 5-fluoro-4-(4-fluoro-2-methoxyphenyl)-N-{4-[(S-methylsulfonimidoyl)methyl] pyridin-2-yl}pyridin-2-amine
Designated 2021-11-12. Orphan designation status: Positive.
First published: 2022-06-09.

Intended use: Treatment of diffuse large B-cell lymphoma

Source: https://www.ema.europa.eu/en/medicines/human/orphan-designations/eu-3-21-2523